EMA orphan designation EU/3/04/210
Active substance: ciclosporin
Orphan designation status: Positive.
First published: 2007-12-13. Last updated: 2019-07-10.

Intended use: Treatment of bronchiolitis obliterans syndrome

Source: https://www.ema.europa.eu/en/medicines/human/orphan-designations/eu-3-04-210